EMA orphan designation EU/3/04/208
Active substance: acetylsalicylic acid
Designated 2004-07-29. Orphan designation status: Withdrawn.
First published: 2004-12-06. Last updated: 2026-05-26.

Intended use: Treatment of polycythaemia vera

Source: https://www.ema.europa.eu/en/medicines/human/orphan-designations/eu-3-04-208